EMA orphan designation EU/3/20/2366
Active substance: autologous CD4+ and CD8+ T cells genetically modified with a lentiviral vector encoding a B-cell maturation antigen-specific chimeric antigen receptor
Designated 2020-12-09. Orphan designation status: Withdrawn.
First published: 2021-05-26.

Intended use: Treatment of multiple myeloma

Source: https://www.ema.europa.eu/en/medicines/human/orphan-designations/eu-3-20-2366